EMA orphan designation EU/3/25/3089
Active substance: methotrexate
Designated 2025-07-18. Orphan designation status: Positive.
First published: 2025-09-04.

Intended use: Treatment of non-syndromic inherited retinal dystrophies of the rod-dominant phenotype

Source: https://www.ema.europa.eu/en/medicines/human/orphan-designations/eu-3-25-3089